EMA orphan designation EU/3/01/031
Active substance: arsenic trioxide
Designated 2001-03-29. Orphan designation status: Withdrawn.
First published: 2007-07-04. Last updated: 2010-03-15.

Intended use: Treatment of myelodysplastic syndromes

Source: https://www.ema.europa.eu/en/medicines/human/orphan-designations/eu-3-01-031